EMA orphan designation EU/3/09/696
Active substance: human MHC non-restricted cytotoxic T-cell line
Designated 2009-11-30. Orphan designation status: Positive.
First published: 2009-12-10. Last updated: 2013-06-19.

Intended use: Treatment of ovarian cancer

Source: https://www.ema.europa.eu/en/medicines/human/orphan-designations/eu-3-09-696